EMA orphan designation EU/3/04/215
Active substance: porfimer sodium
Designated 2004-07-29. Orphan designation status: Withdrawn.
First published: 2004-11-22. Last updated: 2013-04-09.

Intended use: Treatment of cholangiocarcinoma

Source: https://www.ema.europa.eu/en/medicines/human/orphan-designations/eu-3-04-215